EMA orphan designation EU/3/13/1136
Active substance: 5-[1-(2,6-Dichlorobenzyl)piperidin-4-ylmethoxy]quinazoline-2,4-diamine dihydrochloride
Designated 2013-06-07. Orphan designation status: Positive.
First published: 2013-06-25. Last updated: 2013-10-07.

Intended use: Treatment of 5q spinal muscular atrophy

Source: https://www.ema.europa.eu/en/medicines/human/orphan-designations/eu-3-13-1136